EMA orphan designation EU/3/21/2406
Active substance: autologous CD34+ hematopoietic stem and progenitor cells transfected with zinc finger nuclease mRNAs SB-mRENH1 and SB-mRENH2
Designated 2021-02-19. Orphan designation status: Positive.
First published: 2022-02-23. Last updated: 2022-04-25.

Intended use: Treatment of sickle cell disease

Source: https://www.ema.europa.eu/en/medicines/human/orphan-designations/eu-3-21-2406